EMA orphan designation EU/3/23/2778
Active substance: Idronoxil
Designated 2023-05-22. Orphan designation status: Withdrawn.
First published: 2024-01-24.

Intended use: Treatment of soft tissue sarcoma

Source: https://www.ema.europa.eu/en/medicines/human/orphan-designations/eu-3-23-2778